EMA orphan designation EU/3/24/2965
Active substance: 4-(1H-Pyrrolo[2,3-b]pyridin-2-yl)phenol hydrochloride
Designated 2024-08-21. Orphan designation status: Positive.
First published: 2024-09-19.

Intended use: Prevention of acute liver failure

Source: https://www.ema.europa.eu/en/medicines/human/orphan-designations/eu-3-24-2965